EMA orphan designation EU/3/07/450
Active substance: 5(S)-(2'-hydroxy ethoxy)-20(S)-Camptothecin
Designated 2007-06-08. Orphan designation status: Withdrawn.
First published: 2008-07-29. Last updated: 2017-08-14.

Intended use: Treatment of osteosarcoma

Source: https://www.ema.europa.eu/en/medicines/human/orphan-designations/eu-3-07-450